EMA orphan designation EU/3/12/1067
Active substance: Erdosteine
Designated 2012-11-08. Orphan designation status: Positive.
First published: 2012-12-13. Last updated: 2012-12-13.

Intended use: Treatment of mercury toxicity

Source: https://www.ema.europa.eu/en/medicines/human/orphan-designations/eu-3-12-1067